EMA orphan designation EU/3/07/476
Active substance: 5'-O-(trans-9"-octadecenoyl)-1-ß-D-arabinofuranosyl cytosine
Designated 2007-09-14. Orphan designation status: Positive.
First published: 2008-07-29. Last updated: 2014-05-08.

Intended use: Treatment of acute myeloid leukaemia

Source: https://www.ema.europa.eu/en/medicines/human/orphan-designations/eu-3-07-476